EMA orphan designation EU/3/10/840
Active substance: recombinant human histone H1.3 and recombinant human N-bis-met-histone H1.3
Designated 2011-02-23. Orphan designation status: Positive.
First published: 2011-03-07. Last updated: 2013-03-13.

Intended use: Treatment of acute lymphoblastic leukaemia

Source: https://www.ema.europa.eu/en/medicines/human/orphan-designations/eu-3-10-840